EMA orphan designation EU/3/14/1376: Miplyffa
Active substance: arimoclomol citrate
Designated 2014-11-19. Orphan designation status: Positive. EMA product number: EMEA/H/C/005203.
First published: 2015-01-16. Last updated: 2023-04-11.

Intended use: Treatment of Niemann-Pick's disease, type C

Source: https://www.ema.europa.eu/en/medicines/human/orphan-designations/eu-3-14-1376